EMA orphan designation EU/3/04/242: Masipro
Active substance: N-(methyl-diazacyclohexyl-methylbenzamide)-azaphenyl-aminothiopyrrole
Designated 2004-11-16. Orphan designation status: Positive. EMA product number: EMEA/H/C/004159.
First published: 2005-10-27. Last updated: 2005-10-27.

Intended use: Treatment of mastocytosis

Source: https://www.ema.europa.eu/en/medicines/human/orphan-designations/eu-3-04-242